EMA orphan designation EU/3/10/780
Active substance: Forodesine
Designated 2010-09-20. Orphan designation status: Positive.
First published: 2010-10-18. Last updated: 2019-05-21.

Intended use: Treatment of chronic lymphocytic leukaemia

Source: https://www.ema.europa.eu/en/medicines/human/orphan-designations/eu-3-10-780